EMA orphan designation EU/3/13/1158
Active substance: Dexamethasone sodium phosphate encapsulated in human autologous erythrocytes
Designated 2013-07-17. Orphan designation status: Withdrawn.
First published: 2013-08-16. Last updated: 2026-05-18.

Intended use: Treatment of ataxia telangiectasia

Source: https://www.ema.europa.eu/en/medicines/human/orphan-designations/eu-3-13-1158